EMA orphan designation EU/3/11/927
Active substance: sinapultide; Dipalmitoylphosphatidylcholine; palmitoyl-oleoyl phosphatidylglycerol sodium salt; palmitic acid
Designated 2011-10-27. Orphan designation status: Positive.
First published: 2011-11-23. Last updated: 2019-05-22.

Intended use: Treatment of cystic fibrosis

Source: https://www.ema.europa.eu/en/medicines/human/orphan-designations/eu-3-11-927